EMA orphan designation EU/3/10/848
Active substance: sodium thiosulfate
Designated 2011-02-23. Orphan designation status: Withdrawn.
First published: 2011-03-07. Last updated: 2021-02-03.

Intended use: Treatment of calciphylaxis

Source: https://www.ema.europa.eu/en/medicines/human/orphan-designations/eu-3-10-848